EMA orphan designation EU/3/16/1705: Prohippur
Active substance: sodium benzoate
Designated 2016-07-14. Orphan designation status: Positive. EMA product number: EMEA/H/C/004150.
First published: 2016-09-06. Last updated: 2016-09-06.

Intended use: Treatment of ornithine transcarbamylase deficiency

Source: https://www.ema.europa.eu/en/medicines/human/orphan-designations/eu-3-16-1705